EMA orphan designation EU/3/22/2582
Active substance: enzastaurin hydrochloride
Designated 2022-02-24. Orphan designation status: Withdrawn.
First published: 2022-05-13. Last updated: 2024-10-11.

Intended use: Treatment of Ehlers-Danlos syndrome

Source: https://www.ema.europa.eu/en/medicines/human/orphan-designations/eu-3-22-2582